EMA orphan designation EU/3/22/2660
Active substance: panobinostat
Designated 2022-07-18. Orphan designation status: Withdrawn.
First published: 2023-01-23.

Intended use: Treatment of glioma

Source: https://www.ema.europa.eu/en/medicines/human/orphan-designations/eu-3-22-2660